EMA orphan designation EU/3/14/1362
Active substance: 1-(6-benzothiazolylsulfonyl)-5-chloro-1H-indole-2-butanoic acid (lanifibranor)
Designated 2014-11-19. Orphan designation status: Positive.
First published: 2015-01-15. Last updated: 2015-01-15.

Intended use: Treatment of idiopathic pulmonary fibrosis

Source: https://www.ema.europa.eu/en/medicines/human/orphan-designations/eu-3-14-1362